EMA orphan designation EU/3/26/3200
Active substance: aviptadil
Designated 2026-02-23. Orphan designation status: Positive.
First published: 2026-04-14.

Intended use: Treatment of acute respiratory distress syndrome (ARDS)

Source: https://www.ema.europa.eu/en/medicines/human/orphan-designations/eu-3-26-3200